EMA orphan designation EU/3/03/153
Active substance: Herpes simplex virus lacking infected cell protein 34.5
Designated 2003-07-09. Orphan designation status: Positive.
First published: 2003-10-23. Last updated: 2015-03-23.

Intended use: Treatment of glioma

Source: https://www.ema.europa.eu/en/medicines/human/orphan-designations/eu-3-03-153